EMA orphan designation EU/3/14/1375
Active substance: Pro-Pro-Thr-Val-Pro-Thr-Arg
Designated 2014-11-19. Orphan designation status: Withdrawn.
First published: 2015-01-16. Last updated: 2022-02-25.

Intended use: Treatment of xeroderma pigmentosum

Source: https://www.ema.europa.eu/en/medicines/human/orphan-designations/eu-3-14-1375